EMA orphan designation EU/3/18/2055
Designated 2018-08-24. Orphan designation status: Positive.
First published: 2018-12-19.

Intended use: Treatment of glioma

Source: https://www.ema.europa.eu/en/medicines/human/orphan-designations/eu-3-18-2055